EMA orphan designation EU/3/05/303
Active substance: human autologous mesenchymal adult stem cells extracted from adipose tissue
Designated 2005-08-26. Orphan designation status: Withdrawn.
First published: 2009-10-22. Last updated: 2022-07-01.

Intended use: Treatment of anal fistula

Source: https://www.ema.europa.eu/en/medicines/human/orphan-designations/eu-3-05-303